EMA orphan designation EU/3/07/474
Active substance: alpha-1 proteinase inhibitor
Designated 2007-09-14. Orphan designation status: Withdrawn.
First published: 2008-01-17. Last updated: 2020-12-08.

Intended use: Treatment of cystic fibrosis

Source: https://www.ema.europa.eu/en/medicines/human/orphan-designations/eu-3-07-474